EMA orphan designation EU/3/11/912
Active substance: reparixin
Designated 2011-09-27. Orphan designation status: Positive.
First published: 2011-10-17. Last updated: 2015-05-13.

Intended use: Prevention of graft loss in pancreatic islet transplantation

Source: https://www.ema.europa.eu/en/medicines/human/orphan-designations/eu-3-11-912